EMA orphan designation EU/3/22/2572
Active substance: valemetostat tosilate
Designated 2022-02-24. Orphan designation status: Withdrawn.
First published: 2022-04-28.

Intended use: Treatment of peripheral T-cell lymphoma

Source: https://www.ema.europa.eu/en/medicines/human/orphan-designations/eu-3-22-2572